EMA orphan designation EU/3/21/2499
Active substance: Devimistat
Designated 2021-10-15. Orphan designation status: Positive.
First published: 2022-01-20.

Intended use: Treatment of Burkitt's lymphoma

Source: https://www.ema.europa.eu/en/medicines/human/orphan-designations/eu-3-21-2499